EMA orphan designation EU/3/07/499
Active substance: terguride
Designated 2007-11-29. Orphan designation status: Positive.
First published: 2008-07-29. Last updated: 2008-07-29.

Intended use: Treatment of pulmonary arterial hypertension and chronic thromboembolic pulmonary hypertension

Source: https://www.ema.europa.eu/en/medicines/human/orphan-designations/eu-3-07-499